EMA orphan designation EU/3/07/472
Active substance: Cyclo {{(E,Z)-(2S,3R,4R)-3-hydroxy-4-methyl-2-(methylamino)nona-6,8-dienoyl}-L-2-aminobutyryl-N-methyl-glycyl-N-methyl-L-leucyl-L-valyl-N-methyl-L-leucyl-L-alanyl-D-alanyl-N-methyl-L-leucyl-N-methyl-L-leucyl-N-methyl-L-valyl}
Designated 2007-09-14. Orphan designation status: Withdrawn.
First published: 2008-07-29. Last updated: 2013-10-17.

Intended use: Treatment of chronic non-infectious uveitis

Source: https://www.ema.europa.eu/en/medicines/human/orphan-designations/eu-3-07-472